EMA orphan designation EU/3/10/817
Active substance: adeno-associated viral vector containing DNA encoding an RNAi targeting rhodopsin; adeno-associated viral vector containing a rhodopsin gene
Designated 2010-12-17. Orphan designation status: Withdrawn.
First published: 2011-01-19. Last updated: 2019-02-21.

Intended use: Treatment of rhodopsin-linked retinitis pigmentosa

Source: https://www.ema.europa.eu/en/medicines/human/orphan-designations/eu-3-10-817